EMA orphan designation EU/3/16/1814
Active substance: doxorubicin hydrochloride in a lipid-based pegylated nanoparticle modified with a 31-aminoacid peptide targeting nucleolin
Designated 2017-01-12. Orphan designation status: Positive.
First published: 2017-07-14. Last updated: 2017-07-14.

Intended use: Treatment of malignant mesothelioma

Source: https://www.ema.europa.eu/en/medicines/human/orphan-designations/eu-3-16-1814